EMA orphan designation EU/3/16/1816
Active substance: genetically modified adeno-associated viral vector serotype 9 expressing shRNA as well as a codon-optimised shRNA-insensitive wildtype PABPN1
Designated 2017-01-12. Orphan designation status: Positive.
First published: 2017-07-11. Last updated: 2017-07-11.

Intended use: Treatment of oculopharyngeal muscular dystrophy

Source: https://www.ema.europa.eu/en/medicines/human/orphan-designations/eu-3-16-1816